EMA orphan designation EU/3/12/1059
Active substance: milciclib maleate
Designated 2012-11-08. Orphan designation status: Withdrawn.
First published: 2013-07-09. Last updated: 2021-03-22.

Intended use: Treatment of malignant thymoma

Source: https://www.ema.europa.eu/en/medicines/human/orphan-designations/eu-3-12-1059